EMA orphan designation EU/3/16/1753
Active substance: Haematopoietic stem cells modified with a lentiviral vector containing the CD18 gene
Designated 2016-09-20. Orphan designation status: Positive.
First published: 2016-11-15. Last updated: 2022-11-11.

Intended use: Treatment of leukocyte adhesion deficiency type I

Source: https://www.ema.europa.eu/en/medicines/human/orphan-designations/eu-3-16-1753